EMA orphan designation EU/3/04/217
Active substance: sinapultide; Dipalmitoylphosphatidylcholine; palmitoyloleoyl phosphatidylglycerol; palmitic acid
Designated 2004-07-29. Orphan designation status: Positive.
First published: 2009-09-02. Last updated: 2019-05-22.

Intended use: Respiratory distress syndrome in premature neonates of less then 37 weeks of gestational age

Source: https://www.ema.europa.eu/en/medicines/human/orphan-designations/eu-3-04-217